EMA orphan designation EU/3/15/1577
Active substance: adenovirus associated viral vector serotype 5 containing the human RPE65 gene
Designated 2015-11-11. Orphan designation status: Positive.
First published: 2016-01-22. Last updated: 2024-10-22.

Intended use: Treatment of Leber's congenital amaurosis

Source: https://www.ema.europa.eu/en/medicines/human/orphan-designations/eu-3-15-1577